EMA orphan designation EU/3/14/1417
Active substance: A lentiviral vector pseudotyped by the Indiana serotype of the vesicular stomatitis virus G protein encoding an antigen derived from the Tax, HBZ, p12I and p30II HTLV-1 proteins
Designated 2015-01-15. Orphan designation status: Positive.
First published: 2015-03-05. Last updated: 2015-03-05.

Intended use: Treatment of adult T-cell leukaemia/lymphoma

Source: https://www.ema.europa.eu/en/medicines/human/orphan-designations/eu-3-14-1417